EMA orphan designation EU/3/16/1734
Active substance: valproic acid
Designated 2016-08-29. Orphan designation status: Positive.
First published: 2016-10-03. Last updated: 2016-10-03.

Intended use: Treatment of McArdle's disease

Source: https://www.ema.europa.eu/en/medicines/human/orphan-designations/eu-3-16-1734